EMA orphan designation EU/3/24/2921
Active substance: 2,4-Diamino-5-[[5-(1H-pyrazol-5-yl)-2-thienyl]methyl]-1H-pyrimidin-6-one
Designated 2024-05-24. Orphan designation status: Positive.
First published: 2024-08-22.

Intended use: Treatment of hyperphenylalaninaemia

Source: https://www.ema.europa.eu/en/medicines/human/orphan-designations/eu-3-24-2921